EMA orphan designation EU/3/06/377
Active substance: mecasermin rinfabate
Designated 2006-06-20. Orphan designation status: Withdrawn.
First published: 2009-04-02. Last updated: 2009-04-02.

Intended use: Treatment of growth hormone (GH) gene deletion who have developed neutralizing antibodies to GH

Source: https://www.ema.europa.eu/en/medicines/human/orphan-designations/eu-3-06-377